EMA orphan designation EU/3/10/767: Enpaxiq; Epjevy
Active substance: 11-(2-pyrrolidin-1-yl-ethoxy)-14,19-dioxa-5,7,26-triaza-tetracyclo[19.3.1.1(2,6).1(8,12)] heptacosa-1(25),2(26),3,5,8,10,12(27),16,21,23-decaene
Designated 2010-08-25. Orphan designation status: Positive. EMA product number: EMEA/H/C/004193; EMEA/H/C/004793.
First published: 2010-10-18. Last updated: 2024-10-22.

Intended use: Treatment of post-essential thrombocythaemia myelofibrosis

Source: https://www.ema.europa.eu/en/medicines/human/orphan-designations/eu-3-10-767